EMA orphan designation EU/3/11/880
Active substance: genetically modified human adenovirus encoding human PH20 hyaluronidase
Designated 2011-06-21. Orphan designation status: Positive.
First published: 2011-07-12. Last updated: 2013-07-31.

Intended use: Treatment of pancreatic cancer

Source: https://www.ema.europa.eu/en/medicines/human/orphan-designations/eu-3-11-880